EMA orphan designation EU/3/23/2812
Active substance: messenger ribonucleic acid coding for coiled-coil domain-containing protein 40
Designated 2023-07-25. Orphan designation status: Positive.
First published: 2024-02-01.

Intended use: Treatment of primary ciliary dyskinesia

Source: https://www.ema.europa.eu/en/medicines/human/orphan-designations/eu-3-23-2812